EMA orphan designation EU/3/25/3142
Active substance: mezagitamab
Designated 2025-10-20. Orphan designation status: Positive.
First published: 2026-04-14.

Intended use: Treatment of primary IgA nephropathy

Source: https://www.ema.europa.eu/en/medicines/human/orphan-designations/eu-3-25-3142